EMA orphan designation EU/3/17/1871
Active substance: 225Ac-lintuzumab
Designated 2017-05-22. Orphan designation status: Positive.
First published: 2017-06-30. Last updated: 2021-10-13.

Intended use: Treatment of acute myeloid leukaemia

Source: https://www.ema.europa.eu/en/medicines/human/orphan-designations/eu-3-17-1871